EMA orphan designation EU/3/16/1638
Active substance: ubenimex
Designated 2016-03-21. Orphan designation status: Positive.
First published: 2016-05-02. Last updated: 2019-08-19.

Intended use: Treatment of pulmonary arterial hypertension

Source: https://www.ema.europa.eu/en/medicines/human/orphan-designations/eu-3-16-1638